EMA orphan designation EU/3/21/2420: Vijoice
Active substance: alpelisib
Designated 2021-03-26. Orphan designation status: Positive. EMA product number: EMEA/H/C/005468; EMEA/H/C/006539.
First published: 2022-01-19. Last updated: 2026-05-22.

Intended use: Treatment of PIK3CA related overgrowth spectrum

Source: https://www.ema.europa.eu/en/medicines/human/orphan-designations/eu-3-21-2420